EMA orphan designation EU/3/23/2864
Active substance: golcadomide hydrochloride
Designated 2023-12-13. Orphan designation status: Positive.
First published: 2024-02-01.

Intended use: Treatment of follicular lymphoma

Source: https://www.ema.europa.eu/en/medicines/human/orphan-designations/eu-3-23-2864